EMA orphan designation EU/3/08/556
Active substance: N-(2,4-di-tert-butyl-5-hydroxyphenyl)-1,4-dihydro-4-oxoquinoline-3-carboxamide (ivacaftor)
Designated 2008-07-08. Orphan designation status: Expired.
First published: 2009-04-02. Last updated: 2022-08-10.

Intended use: Treatment of cystic fibrosis

Source: https://www.ema.europa.eu/en/medicines/human/orphan-designations/eu-3-08-556